EMA orphan designation EU/3/23/2799
Active substance: humanised IgG1 monoclonal antibody against muscle specific kinase
Designated 2023-07-25. Orphan designation status: Positive.
First published: 2024-01-31.

Intended use: Treatment of congenital myasthenic syndromes

Source: https://www.ema.europa.eu/en/medicines/human/orphan-designations/eu-3-23-2799